EMA orphan designation EU/3/09/661: Xermelo
Active substance: (S)-ethyl 2-amino-3-(4-(2-amino-6((R)-1-(4-chloro-2-(3-methyl-1H-pyrazol-1-yl)phenyl)-2,2,2-trifluoroethoxy)pyrimidin-4-yl)phenyl)propanoate
Orphan designation status: Positive. EMA product number: EMEA/H/C/003937.
First published: 2009-10-20. Last updated: 2022-07-28.

Intended use: Treatment of carcinoid syndrome

Source: https://www.ema.europa.eu/en/medicines/human/orphan-designations/eu-3-09-661